EMA orphan designation EU/3/16/1793
Active substance: zinc gluconate
Designated 2016-11-18. Orphan designation status: Positive.
First published: 2016-12-14. Last updated: 2016-12-14.

Intended use: treatment of facioscapulohumeral muscular dystrophy

Source: https://www.ema.europa.eu/en/medicines/human/orphan-designations/eu-3-16-1793